EMA orphan designation EU/3/23/2882
Active substance: Methyl-(1-{[6-{[(1S)-1-cyclopropylethyl]amino}-2-(pyrazolo[5,1-b][1,3]thiazol-7-yl)-pyrimidin-4-yl]carbonyl}piperidin-4-yl)carbamate mono(4-methylbenzenesulfonate)
Designated 2024-01-12. Orphan designation status: Positive.
First published: 2024-04-11.

Intended use: Treatment of eosinophilic granulomatosis with polyangiitis

Source: https://www.ema.europa.eu/en/medicines/human/orphan-designations/eu-3-23-2882